EMA orphan designation EU/3/21/2565
Active substance: 5' moemC-(sp)-moemC-(p)-moeA-(p)-moemC-(p)-moeG-(p)-moeA-(p)-dmC-(sp)-dA-(sp)-dT-(sp)-dA-(sp)-dT-(sp)-dT-(sp)-dT-(sp)-dT-(sp)-dT-(sp)-dmC-(sp)-moeT-(p)-moeA-(sp)-moemC-(sp)-moeA 3'
Designated 2022-01-14. Orphan designation status: Positive.
First published: 2022-06-09.

Intended use: Treatment of SCN2A developmental and epileptic encephalopathy

Source: https://www.ema.europa.eu/en/medicines/human/orphan-designations/eu-3-21-2565